EMA orphan designation EU/3/09/632
Active substance: adeno-associated viral vector containing porphobilinogen deaminase gene
Designated 2009-04-29. Orphan designation status: Withdrawn.
First published: 2009-05-12. Last updated: 2024-04-09.

Intended use: Treatment of acute intermittent porphyria

Source: https://www.ema.europa.eu/en/medicines/human/orphan-designations/eu-3-09-632